EMA orphan designation EU/3/11/944
Active substance: N,N'-bis(2-mercaptoethyl)isophthalamide (emeramide)
Designated 2012-01-11. Orphan designation status: Positive.
First published: 2012-02-01. Last updated: 2017-08-16.

Intended use: Treatment of mercury toxicity

Source: https://www.ema.europa.eu/en/medicines/human/orphan-designations/eu-3-11-944